EMA orphan designation EU/3/20/2364
Active substance: DNA plasmid encoding human transferrin gene
Designated 2020-11-13. Orphan designation status: Positive.
First published: 2021-02-15.

Intended use: Treatment of retinitis pigmentosa

Source: https://www.ema.europa.eu/en/medicines/human/orphan-designations/eu-3-20-2364